EMA orphan designation EU/3/11/930
Active substance: resminostat
Designated 2011-12-09. Orphan designation status: Withdrawn.
First published: 2011-12-20. Last updated: 2025-06-05.

Intended use: Treatment of Hodgkin's lymphoma

Source: https://www.ema.europa.eu/en/medicines/human/orphan-designations/eu-3-11-930